EMA orphan designation EU/3/19/2207
Active substance: 4-oxo-4H-chromene-2-carboxylic acid (2-(2-4-(2-(6,7-dimethoxy-3,4-dihydro-1H-isoquinolin-2-yl)-ethyl)-phenyl-2H-tetrazol-5-yl)-4,5-dimethoxy-phenyl)-amide (encequidar)
Designated 2019-10-17. Orphan designation status: Positive.
First published: 2020-01-21. Last updated: 2021-09-16.

Intended use: Treatment of soft tissue sarcoma

Source: https://www.ema.europa.eu/en/medicines/human/orphan-designations/eu-3-19-2207